EMA orphan designation EU/3/07/449
Active substance: everolimus
Designated 2007-06-05. Orphan designation status: Withdrawn.
First published: 2007-07-19. Last updated: 2011-09-06.

Intended use: Treatment of renal-cell carcinoma

Source: https://www.ema.europa.eu/en/medicines/human/orphan-designations/eu-3-07-449